EMA orphan designation EU/3/05/294
Active substance: soluble yeast beta-1,3/1,6-glucan
Designated 2005-06-16. Orphan designation status: Positive.
First published: 2005-06-26. Last updated: 2011-04-07.

Intended use: Prevention of oral mucositis in head and neck cancer patients undergoing radiation therapy

Source: https://www.ema.europa.eu/en/medicines/human/orphan-designations/eu-3-05-294